EMA orphan designation EU/3/16/1749
Active substance: crenolanib besilate
Designated 2016-09-20. Orphan designation status: Positive.
First published: 2016-11-15. Last updated: 2016-11-15.

Intended use: Treatment of soft tissue sarcoma

Source: https://www.ema.europa.eu/en/medicines/human/orphan-designations/eu-3-16-1749